EMA orphan designation EU/3/04/251
Active substance: N-(methyl-diazacyclohexyl-methylbenzamide)-azaphenyl-aminothiopyrrole
Designated 2004-12-21. Orphan designation status: Positive.
First published: 2006-01-06. Last updated: 2006-01-06.

Intended use: Treatment of malignant gastrointestinal stromal tumours

Source: https://www.ema.europa.eu/en/medicines/human/orphan-designations/eu-3-04-251